EMA orphan designation EU/3/17/1913
Active substance: teicoplanin
Designated 2017-08-23. Orphan designation status: Positive.
First published: 2017-10-17. Last updated: 2021-05-06.

Intended use: Treatment of cystic fibrosis

Source: https://www.ema.europa.eu/en/medicines/human/orphan-designations/eu-3-17-1913